EMA orphan designation EU/3/14/1382
Active substance: allogeneic CD34+ cells expanded ex vivo with an aryl hydrocarbon receptor antagonist (spanlecortemlocel)
Designated 2014-12-16. Orphan designation status: Withdrawn.
First published: 2015-02-26. Last updated: 2022-09-16.

Intended use: Treatment of acute lymphoblastic leukaemia

Source: https://www.ema.europa.eu/en/medicines/human/orphan-designations/eu-3-14-1382